EMA orphan designation EU/3/18/2089
Active substance: Ex vivo fused normal allogeneic human myoblast with autologous human myoblast derived from Duchenne muscular dystrophy affected donor
Orphan designation status: Positive.
First published: 2019-02-25. Last updated: 2023-04-28.

Intended use: Treatment of Duchenne muscular dystrophy

Source: https://www.ema.europa.eu/en/medicines/human/orphan-designations/eu-3-18-2089